EMA orphan designation EU/3/19/2218
Designated 2019-11-13. Orphan designation status: Positive.
First published: 2020-02-12.

Intended use: Treatment of GM2 gangliosidosis

Source: https://www.ema.europa.eu/en/medicines/human/orphan-designations/eu-3-19-2218